EMA orphan designation EU/3/10/743
Active substance: Pagibaximab
Designated 2010-06-09. Orphan designation status: Withdrawn.
First published: 2010-06-23. Last updated: 2012-02-02.

Intended use: Prevention of sepsis caused by Gram-positive pathogens in premature infants less than or equal to 34

Source: https://www.ema.europa.eu/en/medicines/human/orphan-designations/eu-3-10-743